EMA orphan designation EU/3/10/744
Active substance: adrenomedullin
Designated 2010-06-09. Orphan designation status: Positive.
First published: 2010-06-23. Last updated: 2011-11-18.

Intended use: Treatment of acute lung Injury

Source: https://www.ema.europa.eu/en/medicines/human/orphan-designations/eu-3-10-744